EMA orphan designation EU/3/21/2441
Active substance: adeno-associated viral vector serotype 5 expressing the human Cone-Rod Homeobox gene
Designated 2021-05-20. Orphan designation status: Positive.
First published: 2022-03-18.

Intended use: Treatment of Leber's congenital amaurosis

Source: https://www.ema.europa.eu/en/medicines/human/orphan-designations/eu-3-21-2441